EMA orphan designation EU/3/07/433
Active substance: HLA class I/II binding tumour associated peptides (ADF-APO-CCN-GUC-K67-MET-MMP-MUC-RGS)
Designated 2007-02-15. Orphan designation status: Withdrawn.
First published: 2008-07-10. Last updated: 2016-10-20.

Intended use: Treatment of renal-cell carcinoma

Source: https://www.ema.europa.eu/en/medicines/human/orphan-designations/eu-3-07-433